EMA orphan designation EU/3/17/1900
Active substance: asunercept
Designated 2017-08-23. Orphan designation status: Positive.
First published: 2017-10-17. Last updated: 2023-09-15.

Intended use: Treatment of myelodysplastic syndromes

Source: https://www.ema.europa.eu/en/medicines/human/orphan-designations/eu-3-17-1900